EMA orphan designation EU/3/22/2628
Active substance: 3-(1-(2',3'-dimethoxy-[1,1'-biphenyl]-4-yl)-1H-1,2,3-triazol-4-yl)benzoic acid
Designated 2022-06-21. Orphan designation status: Positive.
First published: 2022-09-15.

Intended use: Treatment of gain-of-function mutations of STIM1 and ORAI1 related diseases

Source: https://www.ema.europa.eu/en/medicines/human/orphan-designations/eu-3-22-2628